EMA orphan designation EU/3/08/607
Active substance: type I native bovine skin collagen
Designated 2009-02-09. Orphan designation status: Positive.
First published: 2009-06-29. Last updated: 2009-06-29.

Intended use: Treatment of systemic sclerosis

Source: https://www.ema.europa.eu/en/medicines/human/orphan-designations/eu-3-08-607